EMA orphan designation EU/3/21/2562
Active substance: Gadolinium-chelated polysiloxane nanoparticles
Designated 2022-01-14. Orphan designation status: Positive.
First published: 2022-06-09.

Intended use: Treatment of glioma

Source: https://www.ema.europa.eu/en/medicines/human/orphan-designations/eu-3-21-2562